EMA orphan designation EU/3/13/1121
Active substance: lenvatinib
Designated 2013-04-26. Orphan designation status: Withdrawn.
First published: 2013-05-14. Last updated: 2018-10-22.

Intended use: Treatment of papillary thyroid cancer

Source: https://www.ema.europa.eu/en/medicines/human/orphan-designations/eu-3-13-1121